EMA orphan designation EU/3/00/004
Active substance: Fluorouracil
Designated 2000-10-18. Orphan designation status: Withdrawn.
First published: 2009-05-15. Last updated: 2009-05-15.

Intended use: Treatment of glioblastoma

Source: https://www.ema.europa.eu/en/medicines/human/orphan-designations/eu-3-00-004